EMA orphan designation EU/3/09/633
Active substance: L-asparaginase encapsulated in erythrocytes
Designated 2009-05-15. Orphan designation status: Positive.
First published: 2009-06-09. Last updated: 2009-06-09.

Intended use: Treatment of pancreatic cancer

Source: https://www.ema.europa.eu/en/medicines/human/orphan-designations/eu-3-09-633